EMA orphan designation EU/3/13/1126
Active substance: Mexiletine hydrochloride
Designated 2013-06-07. Orphan designation status: Positive.
First published: 2013-06-25. Last updated: 2021-04-30.

Intended use: Treatment of non-dystrophic myotonia

Source: https://www.ema.europa.eu/en/medicines/human/orphan-designations/eu-3-13-1126